EMA orphan designation EU/3/24/3001
Active substance: votoplam
Designated 2024-12-13. Orphan designation status: Positive.
First published: 2025-01-13.

Intended use: Treatment of Huntington's disease

Source: https://www.ema.europa.eu/en/medicines/human/orphan-designations/eu-3-24-3001